EMA orphan designation EU/3/22/2741
Active substance: autologous hematopoietic cells genetically modified with a lentiviral vector containing the human RAG2 gene
Designated 2023-01-13. Orphan designation status: Positive.
First published: 2023-03-23.

Intended use: Treatment of recombination-activating gene 2 deficient severe combined immunodeficiency

Source: https://www.ema.europa.eu/en/medicines/human/orphan-designations/eu-3-22-2741